EMA orphan designation EU/3/21/2501
Active substance: batiraxcept
Designated 2021-10-15. Orphan designation status: Withdrawn.
First published: 2022-01-20.

Intended use: Treatment of ovarian cancer

Source: https://www.ema.europa.eu/en/medicines/human/orphan-designations/eu-3-21-2501